EMA orphan designation EU/3/14/1248
Active substance: N-({Carbamoylmethyl-[3-(2-oxo-pyrrolidin-1-yl)-propyl]-carbamoyl}-methyl)-2-[2-(2-fluoro-phenyl)-ethylamino]-N-isobutyl-acetamide
Designated 2014-03-31. Orphan designation status: Positive.
First published: 2014-04-03. Last updated: 2022-10-31.

Intended use: Treatment of optic neuritis

Source: https://www.ema.europa.eu/en/medicines/human/orphan-designations/eu-3-14-1248